EMA orphan designation EU/3/23/2774
Active substance: lurbinectedin
Designated 2023-04-21. Orphan designation status: Positive.
First published: 2024-01-31.

Intended use: Treatment of soft tissue sarcoma

Source: https://www.ema.europa.eu/en/medicines/human/orphan-designations/eu-3-23-2774